EMA orphan designation EU/3/16/1801
Active substance: Ibudilast
Designated 2016-12-12. Orphan designation status: Positive.
First published: 2017-01-09. Last updated: 2019-10-16.

Intended use: Treatment of amyotrophic lateral sclerosis

Source: https://www.ema.europa.eu/en/medicines/human/orphan-designations/eu-3-16-1801